EMA orphan designation EU/3/16/1709
Active substance: sodium hypochlorite
Designated 2016-07-14. Orphan designation status: Positive.
First published: 2016-09-06. Last updated: 2016-09-06.

Intended use: Treatment of partial deep dermal and full-thickness burns

Source: https://www.ema.europa.eu/en/medicines/human/orphan-designations/eu-3-16-1709